EMA orphan designation EU/3/17/1959: Epidyolex
Active substance: cannabidiol
Designated 2018-01-17. Orphan designation status: Positive. EMA product number: EMEA/H/C/004675.
First published: 2018-03-12. Last updated: 2023-06-15.

Intended use: Treatment of tuberous sclerosis

Source: https://www.ema.europa.eu/en/medicines/human/orphan-designations/eu-3-17-1959